EMA orphan designation EU/3/24/2947
Active substance: afamelanotide
Designated 2024-07-25. Orphan designation status: Positive.
First published: 2024-09-13.

Intended use: Treatment of variegate porphyria

Source: https://www.ema.europa.eu/en/medicines/human/orphan-designations/eu-3-24-2947